EMA orphan designation EU/3/20/2262
Active substance: fosgemcitabine palabenamide
Designated 2020-03-24. Orphan designation status: Withdrawn.
First published: 2020-08-12.

Intended use: Treatment of biliary tract cancer

Source: https://www.ema.europa.eu/en/medicines/human/orphan-designations/eu-3-20-2262